EMA orphan designation EU/3/15/1519
Active substance: allogeneic human adult stem cells, isolated from skeletal muscle and expanded ex vivo
Designated 2015-07-28. Orphan designation status: Positive.
First published: 2015-08-18. Last updated: 2015-08-18.

Intended use: Treatment of Duchenne muscular dystrophy

Source: https://www.ema.europa.eu/en/medicines/human/orphan-designations/eu-3-15-1519